EMA orphan designation EU/3/23/2880
Active substance: adenine
Designated 2024-01-12. Orphan designation status: Positive.
First published: 2024-04-11.

Intended use: Treatment of epidermolysis bullosa

Source: https://www.ema.europa.eu/en/medicines/human/orphan-designations/eu-3-23-2880